EMA orphan designation EU/3/18/1976
Active substance: allogeneic CD4+ and CD25+ T lymphocytes ex vivo incubated with GP120
Designated 2018-02-22. Orphan designation status: Positive.
First published: 2018-04-11. Last updated: 2021-09-17.

Intended use: Treatment in haematopoietic stem cell transplantation

Source: https://www.ema.europa.eu/en/medicines/human/orphan-designations/eu-3-18-1976